EMA orphan designation EU/3/17/1856
Active substance: inebilizumab
Designated 2017-03-20. Orphan designation status: Withdrawn.
First published: 2017-05-05. Last updated: 2022-05-19.

Intended use: Treatment of neuromyelitis optica spectrum disorders

Source: https://www.ema.europa.eu/en/medicines/human/orphan-designations/eu-3-17-1856